EMA orphan designation EU/3/07/460
Active substance: arsenic trioxide
Designated 2007-08-02. Orphan designation status: Withdrawn.
First published: 2008-07-10. Last updated: 2010-03-15.

Intended use: Treatment of acute myeloid leukaemia

Source: https://www.ema.europa.eu/en/medicines/human/orphan-designations/eu-3-07-460